EMA orphan designation EU/3/14/1411
Active substance: pentosan polysulfate sodium
Designated 2015-01-15. Orphan designation status: Withdrawn.
First published: 2015-03-05. Last updated: 2016-02-09.

Intended use: Treatment of interstitial cystitis

Source: https://www.ema.europa.eu/en/medicines/human/orphan-designations/eu-3-14-1411